EMA orphan designation EU/3/13/1213
Active substance: Lactobacillus acidophilus and Bifidobacterium bifidum
Designated 2014-01-16. Orphan designation status: Positive.
First published: 2014-01-23. Last updated: 2014-01-23.

Intended use: Prevention of necrotising enterocolitis

Source: https://www.ema.europa.eu/en/medicines/human/orphan-designations/eu-3-13-1213